EMA orphan designation EU/3/24/2905
Active substance: autologous CD3-positive T-cells expressing a chimeric antigen receptor against B cell maturation agent
Designated 2024-03-21. Orphan designation status: Positive.
First published: 2024-07-23.

Intended use: Treatment of multiple myeloma

Source: https://www.ema.europa.eu/en/medicines/human/orphan-designations/eu-3-24-2905